EMA orphan designation EU/3/09/625
Active substance: Guanabenz
Designated 2009-04-29. Orphan designation status: Positive.
First published: 2009-05-12. Last updated: 2009-05-12.

Intended use: Treatment of traumatic spinal cord injury

Source: https://www.ema.europa.eu/en/medicines/human/orphan-designations/eu-3-09-625